EMA orphan designation EU/3/17/1956
Active substance: adeno-associated viral vector serotype 2/6 encoding zinc-finger nucleases and the human iduronate 2-sulfatase gene
Designated 2018-01-17. Orphan designation status: Withdrawn.
First published: 2018-03-13. Last updated: 2023-09-12.

Intended use: Treatment of mucopolysaccharidosis type II (Hunter's syndrome)

Source: https://www.ema.europa.eu/en/medicines/human/orphan-designations/eu-3-17-1956